EMA orphan designation EU/3/22/2671
Active substance: autologous CD34+ haematopoietic stem and progenitor cells genetically modified with a lentiviral vector encoding for the N-acetylgalactosamine 6-sulfatase cDNA
Designated 2022-08-10. Orphan designation status: Positive.
First published: 2022-12-15.

Intended use: Treatment of mucopolysaccharidosis type IV A (Morquio A syndrome)

Source: https://www.ema.europa.eu/en/medicines/human/orphan-designations/eu-3-22-2671